EMA orphan designation EU/3/02/123
Active substance: anti-CD 147 murine monoclonal IgM
Designated 2002-11-14. Orphan designation status: Withdrawn.
First published: 2003-07-07. Last updated: 2003-07-07.

Intended use: Treatment of steroid resistant graft versus host disease (GvHD)

Source: https://www.ema.europa.eu/en/medicines/human/orphan-designations/eu-3-02-123